EMA orphan designation EU/3/08/606
Active substance: recombinant human residue 41 glutamic acid to glutamine variant of interferon alfa-2b
Designated 2009-01-19. Orphan designation status: Withdrawn.
First published: 2009-06-29. Last updated: 2011-05-18.

Intended use: Treatment of Behçet's Disease

Source: https://www.ema.europa.eu/en/medicines/human/orphan-designations/eu-3-08-606